EMA orphan designation EU/3/21/2459: Redemplo
Active substance: synthetic double-stranded siRNA oligonucleotide directed against apolipoprotein C-III mRNA and covalently linked to a ligand containing three N-acetylgalactosamine residues
Designated 2021-07-19. Orphan designation status: Positive. EMA product number: EMEA/H/C/006579.
First published: 2021-11-12. Last updated: 2026-04-24.

Intended use: Treatment of familial chylomicronaemia syndrome

Source: https://www.ema.europa.eu/en/medicines/human/orphan-designations/eu-3-21-2459